EMA orphan designation EU/3/23/2884
Active substance: adeno-associated viral vector serotype 9 containing the human MECP2 gene, an intron encoding a miRNA generating sequence, and complementary miRNA binding sites
Designated 2024-01-12. Orphan designation status: Positive.
First published: 2024-04-11.

Intended use: Treatment of Rett syndrome

Source: https://www.ema.europa.eu/en/medicines/human/orphan-designations/eu-3-23-2884